EMA orphan designation EU/3/19/2161
Active substance: Diacerein
Designated 2019-05-29. Orphan designation status: Positive.
First published: 2019-08-09. Last updated: 2019-08-19.

Intended use: Treatment of epidermolysis bullosa

Source: https://www.ema.europa.eu/en/medicines/human/orphan-designations/eu-3-19-2161